EMA orphan designation EU/3/23/2748
Active substance: Fosmanogepix
Designated 2023-02-15. Orphan designation status: Positive.
First published: 2024-01-25. Last updated: 2024-10-15.

Intended use: Treatment of fusariosis

Source: https://www.ema.europa.eu/en/medicines/human/orphan-designations/eu-3-23-2748